EMA orphan designation EU/3/02/115: Winfuran
Designated 2002-09-11. Orphan designation status: Positive. EMA product number: EMEA/H/C/002683.
First published: 2009-02-10. Last updated: 2017-02-14.

Intended use: Treatment of uraemic pruritus

Source: https://www.ema.europa.eu/en/medicines/human/orphan-designations/eu-3-02-115